EMA orphan designation EU/3/19/2212
Active substance: leriglitazone
Designated 2019-10-17. Orphan designation status: Positive.
First published: 2020-01-22.

Intended use: Treatment of Friedreich’s ataxia

Source: https://www.ema.europa.eu/en/medicines/human/orphan-designations/eu-3-19-2212